EMA orphan designation EU/3/24/2983
Active substance: Interleukin-12, human, recombinant
Designated 2024-11-10. Orphan designation status: Positive.
First published: 2024-11-20.

Intended use: Treatment of cutaneous T-cell lymphoma

Source: https://www.ema.europa.eu/en/medicines/human/orphan-designations/eu-3-24-2983